EMA orphan designation EU/3/14/1428
Active substance: sodium valproate fNor
Designated 2015-01-15. Orphan designation status: Positive.
First published: 2015-03-06. Last updated: 2021-09-16.

Intended use: Treatment of Wolfram syndrome

Source: https://www.ema.europa.eu/en/medicines/human/orphan-designations/eu-3-14-1428